EMA orphan designation EU/3/15/1463
Active substance: Melphalan flufenamide
Designated 2015-03-19. Orphan designation status: Withdrawn.
First published: 2015-05-11. Last updated: 2022-08-22.

Intended use: Treatment of plasma cell myeloma

Source: https://www.ema.europa.eu/en/medicines/human/orphan-designations/eu-3-15-1463